EMA orphan designation EU/3/08/576
Active substance: carglumic acid
Designated 2008-11-07. Orphan designation status: Expired.
First published: 2009-04-02. Last updated: 2021-06-09.

Intended use: Treatment of methylmalonic acidaemia

Source: https://www.ema.europa.eu/en/medicines/human/orphan-designations/eu-3-08-576